EMA orphan designation EU/3/07/456
Active substance: rilonacept
Designated 2007-07-10. Orphan designation status: Withdrawn.
First published: 2008-07-29. Last updated: 2019-02-21.

Intended use: Treatment of cryopirin-associated periodic syndromes

Source: https://www.ema.europa.eu/en/medicines/human/orphan-designations/eu-3-07-456